EMA orphan designation EU/3/25/3158
Active substance: resecabtagene autoleucel
Designated 2025-11-21. Orphan designation status: Positive.
First published: 2026-04-14.

Intended use: Treatment of systemic sclerosis

Source: https://www.ema.europa.eu/en/medicines/human/orphan-designations/eu-3-25-3158